EMA orphan designation EU/3/16/1678: Luxceptar
Active substance: Donor T lymphocytes depleted ex vivo of host alloreactive T cells using photodynamic treatment-
Designated 2016-06-27. Orphan designation status: Withdrawn. EMA product number: EMEA/H/C/002397.
First published: 2016-07-26. Last updated: 2022-02-28.

Intended use: Treatment in haematopoietic stem cell transplantation

Source: https://www.ema.europa.eu/en/medicines/human/orphan-designations/eu-3-16-1678